EMA orphan designation EU/3/25/3124
Active substance: mezagitamab
Designated 2025-08-22. Orphan designation status: Positive.
First published: 2026-04-10.

Intended use: Treatment of immune thrombocytopenia

Source: https://www.ema.europa.eu/en/medicines/human/orphan-designations/eu-3-25-3124